EMA orphan designation EU/3/20/2258
Active substance: sutimlimab
Designated 2020-02-28. Orphan designation status: Withdrawn.
First published: 2020-05-06. Last updated: 2026-05-26.

Intended use: Treatment of immune thrombocytopenia

Source: https://www.ema.europa.eu/en/medicines/human/orphan-designations/eu-3-20-2258